EMA orphan designation EU/3/14/1399
Active substance: edaravone
Designated 2014-12-16. Orphan designation status: Positive.
First published: 2015-02-26. Last updated: 2024-10-31.

Intended use: Treatment of amyotrophic lateral sclerosis

Source: https://www.ema.europa.eu/en/medicines/human/orphan-designations/eu-3-14-1399